EMA orphan designation EU/3/18/2024
Active substance: Itraconazole
Designated 2018-05-25. Orphan designation status: Positive.
First published: 2018-07-24. Last updated: 2020-05-15.

Intended use: Prevention of invasive aspergillosis

Source: https://www.ema.europa.eu/en/medicines/human/orphan-designations/eu-3-18-2024